EMA orphan designation EU/3/09/703
Active substance: brivudine
Designated 2010-01-28. Orphan designation status: Positive.
First published: 2010-02-25. Last updated: 2010-02-25.

Intended use: Treatment of pancreatic cancer

Source: https://www.ema.europa.eu/en/medicines/human/orphan-designations/eu-3-09-703